EMA orphan designation EU/3/09/715
Active substance: Benzamide, 3-(2-imidazo[1,2-b]pyridazin-3-ylethynyl)-4-methyl-N-[4-[(4-methyl-1-piperazinyl)methyl]-3-(trifluoromethyl)phenyl] (ponatinib)
Designated 2010-02-02. Orphan designation status: Expired.
First published: 2010-03-04. Last updated: 2023-07-20.

Intended use: Treatment of acute lymphoblastic leukaemia

Source: https://www.ema.europa.eu/en/medicines/human/orphan-designations/eu-3-09-715